EMA orphan designation EU/3/17/1915
Active substance: 2-[N-(2-hydroxyethyl)]-N-(4-methoxybenzenesulfonyl)]amino-N-(4-chlorocinnamyl)-N-methylbenzylamine
Designated 2017-10-16. Orphan designation status: Positive.
First published: 2018-01-08. Last updated: 2018-01-08.

Intended use: Treatment of Charcot-Marie-Tooth disease

Source: https://www.ema.europa.eu/en/medicines/human/orphan-designations/eu-3-17-1915